EMA orphan designation EU/3/23/2755
Active substance: adeno-associated viral vector serotype 9 expressing fukutin-related protein
Designated 2023-02-15. Orphan designation status: Positive.
First published: 2024-01-25.

Intended use: Treatment of limb girdle muscular dystrophy

Source: https://www.ema.europa.eu/en/medicines/human/orphan-designations/eu-3-23-2755